EMA orphan designation EU/3/16/1765
Active substance: ubiquinol
Designated 2016-09-20. Orphan designation status: Positive.
First published: 2016-11-15. Last updated: 2022-11-11.

Intended use: Treatment of primary coenzyme Q10 deficiency syndrome

Source: https://www.ema.europa.eu/en/medicines/human/orphan-designations/eu-3-16-1765